EMA orphan designation EU/3/20/2296
Active substance: axicabtagene ciloleucel
Designated 2020-06-26. Orphan designation status: Positive.
First published: 2020-09-24. Last updated: 2021-09-15.

Intended use: Treatment of marginal zone lymphoma

Source: https://www.ema.europa.eu/en/medicines/human/orphan-designations/eu-3-20-2296